EMA orphan designation EU/3/26/3203
Active substance: spironolactone
Designated 2026-02-23. Orphan designation status: Positive.
First published: 2026-04-10.

Intended use: Treatment of limbal stem cell deficiency

Source: https://www.ema.europa.eu/en/medicines/human/orphan-designations/eu-3-26-3203